EMA orphan designation EU/3/15/1593
Active substance: imetelstat sodium
Designated 2015-12-14. Orphan designation status: Positive.
First published: 2016-02-03. Last updated: 2023-06-16.

Intended use: Treatment of myelofibrosis

Source: https://www.ema.europa.eu/en/medicines/human/orphan-designations/eu-3-15-1593